EMA orphan designation EU/3/09/688
Active substance: patupilone
Designated 2009-11-05. Orphan designation status: Withdrawn.
First published: 2009-11-18. Last updated: 2011-03-29.

Intended use: Treatment of fallopian tube cancer

Source: https://www.ema.europa.eu/en/medicines/human/orphan-designations/eu-3-09-688